EMA orphan designation EU/3/09/631
Active substance: talampanel
Designated 2009-04-29. Orphan designation status: Withdrawn.
First published: 2009-05-12. Last updated: 2011-05-18.

Intended use: Treatment of glioma

Source: https://www.ema.europa.eu/en/medicines/human/orphan-designations/eu-3-09-631